EMA orphan designation EU/3/24/2912
Active substance: autologous adipose-derived mesenchymal stem cells embedded in an extracellular matrix with hydroxyapatite/beta-tricalcium phosphate particles
Designated 2024-04-16. Orphan designation status: Positive.
First published: 2024-07-26.

Intended use: Treatment of congenital pseudarthrosis of long bones

Source: https://www.ema.europa.eu/en/medicines/human/orphan-designations/eu-3-24-2912